EMA orphan designation EU/3/13/1108
Active substance: 2-[4-Methoxy-3-(2-m-tolyl-ethoxy)-benzoylamino]-indan-2-carboxylic acid
Designated 2013-03-12. Orphan designation status: Withdrawn.
First published: 2013-04-05. Last updated: 2026-05-18.

Intended use: Treatment of systemic sclerosis

Source: https://www.ema.europa.eu/en/medicines/human/orphan-designations/eu-3-13-1108